EMA orphan designation EU/3/11/941
Active substance: Lipopolysaccharide of Ochrobactrum intermedium
Designated 2012-01-11. Orphan designation status: Positive.
First published: 2012-02-01. Last updated: 2014-10-08.

Intended use: Prevention of sepsis in at-risk premature infants of less than or equal to 32 weeks

Source: https://www.ema.europa.eu/en/medicines/human/orphan-designations/eu-3-11-941